EMA orphan designation EU/3/19/2203
Active substance: (S)-2-isobutyrylamino-pentanedioic acid 5-amide 1-{[(2S,5S,8S,11R,12S,15S,18S,21R)-2,8-bis-((S)-sec-butyl)-21-hydroxy-5-(4-hydroxy-benzyl)-15-isobutyl-4,11-dimethyl-3,6,9,13,16,22-hexaoxo-10-oxa-1,4,7,14,17-pentaaza-bicyclo[16.3.1]docos-12-yl]-amide}
Designated 2019-10-17. Orphan designation status: Withdrawn.
First published: 2020-01-21. Last updated: 2025-06-30.

Intended use: Treatment of Netherton syndrome

Source: https://www.ema.europa.eu/en/medicines/human/orphan-designations/eu-3-19-2203